EMA orphan designation EU/3/08/554
Active substance: beraprost sodium
Designated 2008-07-10. Orphan designation status: Withdrawn.
First published: 2009-04-24. Last updated: 2020-02-11.

Intended use: Treatment of pulmonary arterial hypertension

Source: https://www.ema.europa.eu/en/medicines/human/orphan-designations/eu-3-08-554